EMA orphan designation EU/3/12/1096
Active substance: terguride
Designated 2013-01-24. Orphan designation status: Withdrawn.
First published: 2013-03-01. Last updated: 2021-03-22.

Intended use: Treatment of systemic sclerosis

Source: https://www.ema.europa.eu/en/medicines/human/orphan-designations/eu-3-12-1096